EMA orphan designation EU/3/20/2362
Active substance: autologous bone marrow derived CD34+ cells transduced ex vivo with a self-inactivating lentiviral vector containing a normal version of the coding region of the IL2RG gene
Designated 2020-11-13. Orphan designation status: Withdrawn.
First published: 2021-02-15. Last updated: 2024-11-19.

Intended use: Treatment of X-linked severe combined immunodeficiency

Source: https://www.ema.europa.eu/en/medicines/human/orphan-designations/eu-3-20-2362